EMA orphan designation EU/3/22/2614
Active substance: elamipretide
Designated 2022-05-16. Orphan designation status: Positive.
First published: 2022-12-14.

Intended use: Treatment of myopathic mitochondrial DNA depletion syndrome

Source: https://www.ema.europa.eu/en/medicines/human/orphan-designations/eu-3-22-2614